EMA orphan designation EU/3/23/2788
Active substance: adeno-associated virus vector serotype 8 encoding the ABCA4 protein, C-region, adeno-associated virus vector serotype 8 encoding the ABCA4 protein, N-region
Designated 2023-06-20. Orphan designation status: Positive.
First published: 2024-02-02.

Intended use: Treatment of inherited retinal dystrophies

Source: https://www.ema.europa.eu/en/medicines/human/orphan-designations/eu-3-23-2788